EMA orphan designation EU/3/15/1442
Active substance: fibrinogen-coated albumin spheres
Designated 2015-02-12. Orphan designation status: Positive.
First published: 2015-04-09. Last updated: 2019-05-22.

Intended use: Treatment of Ebola virus disease

Source: https://www.ema.europa.eu/en/medicines/human/orphan-designations/eu-3-15-1442